EMA orphan designation EU/3/21/2492
Active substance: adeno-associated virus serotype 9 encoding human NGLY1 gene
Designated 2021-08-20. Orphan designation status: Positive.
First published: 2022-03-03.

Intended use: Treatment of NGLY1 deficiency

Source: https://www.ema.europa.eu/en/medicines/human/orphan-designations/eu-3-21-2492